EMA orphan designation EU/3/25/3167
Active substance: zimberelimab
Designated 2025-09-12. Orphan designation status: Positive.
First published: 2026-03-26.

Intended use: Treatment of gastric cancer

Source: https://www.ema.europa.eu/en/medicines/human/orphan-designations/eu-3-25-3167